EMA orphan designation EU/3/05/320
Active substance: imatinib mesilate
Designated 2005-10-28. Orphan designation status: Expired.
First published: 2007-02-28. Last updated: 2015-02-09.

Intended use: Treatment of chronic eosinophilic leukaemia and the hypereosinophilic syndrome

Source: https://www.ema.europa.eu/en/medicines/human/orphan-designations/eu-3-05-320